EMA orphan designation EU/3/21/2510
Active substance: encaleret
Designated 2021-10-15. Orphan designation status: Positive.
First published: 2022-01-21. Last updated: 2026-05-11.

Intended use: Treatment of hypoparathyroidism

Source: https://www.ema.europa.eu/en/medicines/human/orphan-designations/eu-3-21-2510